EMA orphan designation EU/3/19/2165
Active substance: sodium phenylacetate; sodium benzoate
Designated 2019-05-29. Orphan designation status: Positive.
First published: 2019-08-12.

Intended use: Treatment of citrullinaemia type 1

Source: https://www.ema.europa.eu/en/medicines/human/orphan-designations/eu-3-19-2165